EMA orphan designation EU/3/12/1072
Active substance: Encapsulated human retinal pigment epithelial cell line transfected with plasmid vector expressing human ciliary neurotropic factor
Designated 2012-11-08. Orphan designation status: Positive.
First published: 2012-12-12. Last updated: 2022-04-12.

Intended use: Treatment of macular telangiectasia type 2

Source: https://www.ema.europa.eu/en/medicines/human/orphan-designations/eu-3-12-1072